EMA orphan designation EU/3/21/2547
Active substance: chimeric peptide of human glucagon-like peptide-1, glucagon and gastric inhibitory polypeptide analogues linked to a human immunoglobulin Fc fragment
Designated 2021-12-10. Orphan designation status: Positive.
First published: 2022-04-28.

Intended use: Treatment of primary sclerosing cholangitis

Source: https://www.ema.europa.eu/en/medicines/human/orphan-designations/eu-3-21-2547